EMA orphan designation EU/3/03/161
Active substance: antisense oligonucleotide (TATCCGGAGGGCTCGCCATGCTGCT)
Designated 2003-10-02. Orphan designation status: Positive.
First published: 2004-04-26. Last updated: 2004-04-26.

Intended use: Treatment of neovascular glaucoma

Source: https://www.ema.europa.eu/en/medicines/human/orphan-designations/eu-3-03-161